EMA orphan designation EU/3/08/566
Active substance: Levofloxacin hemihydrate
Designated 2008-09-23. Orphan designation status: Withdrawn.
First published: 2009-04-24. Last updated: 2018-02-09.

Intended use: Treatment of cystic fibrosis

Source: https://www.ema.europa.eu/en/medicines/human/orphan-designations/eu-3-08-566